EMA orphan designation EU/3/12/1051
Active substance: recombinant human lecithin cholesterol acyltransferase
Designated 2012-10-10. Orphan designation status: Withdrawn.
First published: 2012-11-16. Last updated: 2016-08-15.

Intended use: Treatment of lecithin-cholesterol-acyltransferase deficiency

Source: https://www.ema.europa.eu/en/medicines/human/orphan-designations/eu-3-12-1051